EMA orphan designation EU/3/13/1212
Active substance: Ibrutinib
Designated 2014-01-16. Orphan designation status: Withdrawn.
First published: 2014-01-23. Last updated: 2021-11-23.

Intended use: Treatment of follicular lymphoma

Source: https://www.ema.europa.eu/en/medicines/human/orphan-designations/eu-3-13-1212